EMA orphan designation EU/3/13/1175
Active substance: tolvaptan
Designated 2013-08-05. Orphan designation status: Withdrawn.
First published: 2013-09-13. Last updated: 2018-02-12.

Intended use: Treatment of autosomal dominant polycystic kidney disease

Source: https://www.ema.europa.eu/en/medicines/human/orphan-designations/eu-3-13-1175